EMA orphan designation EU/3/09/629: Cablivi
Active substance: nanobody directed towards the human A1 domain of von Willebrand factor (caplacizumab)
Designated 2009-04-30. Orphan designation status: Positive. EMA product number: EMEA/H/C/004426.
First published: 2009-05-12. Last updated: 2018-09-10.

Intended use: Treatment of thrombotic thrombocytopenic purpura

Source: https://www.ema.europa.eu/en/medicines/human/orphan-designations/eu-3-09-629